EMA orphan designation EU/3/07/438
Active substance: zanolimumab
Designated 2007-03-20. Orphan designation status: Positive.
First published: 2008-08-18. Last updated: 2011-03-10.

Intended use: Treatment of peripheral T-cell lymphoma (nodal, other extranodal and leukaemic/disseminated)

Source: https://www.ema.europa.eu/en/medicines/human/orphan-designations/eu-3-07-438